EMA orphan designation EU/3/13/1152
Active substance: (1-methyl-2-nitro-1H-imidazole-5-yl)methyl N,N'-bis(2-bromoethyl)diamidophosphate
Designated 2013-07-17. Orphan designation status: Withdrawn.
First published: 2013-08-16. Last updated: 2017-10-19.

Intended use: Treatment of pancreatic cancer

Source: https://www.ema.europa.eu/en/medicines/human/orphan-designations/eu-3-13-1152